EMA orphan designation EU/3/04/190
Active substance: Ethanol
Designated 2004-03-09. Orphan designation status: Withdrawn.
First published: 2005-04-28. Last updated: 2016-01-14.

Intended use: Treatment of congenital lymphatic malformations

Source: https://www.ema.europa.eu/en/medicines/human/orphan-designations/eu-3-04-190